EMA orphan designation EU/3/02/128: Voraxaze
Active substance: carboxypeptidase G2 (glucarpidase)
Designated 2003-02-03. Orphan designation status: Positive. EMA product number: EMEA/H/C/000681; EMEA/H/C/005467.
First published: 2003-02-17. Last updated: 2022-01-20.

Intended use: Adjunctive treatment in patients at risk of methotrexate toxicity

Source: https://www.ema.europa.eu/en/medicines/human/orphan-designations/eu-3-02-128